EMA orphan designation EU/3/25/3091
Active substance: allogeneic peripheral blood-derived T-cells, fratricide-resistant, transduced with a lentivirus vector expressing a chimeric antigen receptor against CD7
Designated 2025-07-18. Orphan designation status: Positive.
First published: 2025-09-04.

Intended use: Treatment of acute lymphoblastic leukaemia

Source: https://www.ema.europa.eu/en/medicines/human/orphan-designations/eu-3-25-3091